EMA orphan designation EU/3/16/1809
Active substance: [5,10,15,20-tetrakis(4-carboxyphenyl)-21H,23H-porphine]manganese(III) chloride
Designated 2017-01-12. Orphan designation status: Positive.
First published: 2017-07-13. Last updated: 2017-07-13.

Intended use: Treatment of Cockayne syndrome

Source: https://www.ema.europa.eu/en/medicines/human/orphan-designations/eu-3-16-1809